EMA orphan designation EU/3/09/648
Active substance: afamelanotide
Designated 2009-07-24. Orphan designation status: Positive.
First published: 2009-09-11. Last updated: 2016-04-11.

Intended use: Treatment of solar urticaria

Source: https://www.ema.europa.eu/en/medicines/human/orphan-designations/eu-3-09-648